EMA orphan designation EU/3/15/1490
Active substance: triamcinolone acetonide
Designated 2015-05-21. Orphan designation status: Positive.
First published: 2015-06-30. Last updated: 2023-06-09.

Intended use: Treatment of non-infectious uveitis

Source: https://www.ema.europa.eu/en/medicines/human/orphan-designations/eu-3-15-1490